EMA orphan designation EU/3/22/2730
Active substance: Duvelisib
Designated 2022-12-09. Orphan designation status: Positive.
First published: 2023-03-13.

Intended use: Treatment of peripheral T-cell lymphoma

Source: https://www.ema.europa.eu/en/medicines/human/orphan-designations/eu-3-22-2730